EMA orphan designation EU/3/22/2621
Active substance: Escherichia coli, strain Nissle 1917, expressing high affinity phenylalanine transporter, phenylalanine ammonia lyase and L-amino acid deaminase
Designated 2022-06-21. Orphan designation status: Withdrawn.
First published: 2022-09-15.

Intended use: Treatment of hyperphenylalaninaemia

Source: https://www.ema.europa.eu/en/medicines/human/orphan-designations/eu-3-22-2621